EMA orphan designation EU/3/08/584
Active substance: palifosfamide
Designated 2008-12-03. Orphan designation status: Withdrawn.
First published: 2009-10-30. Last updated: 2016-01-14.

Intended use: Treatment of soft tissue sarcoma

Source: https://www.ema.europa.eu/en/medicines/human/orphan-designations/eu-3-08-584